EMA orphan designation EU/3/11/936
Active substance: human haptoglobin
Designated 2011-12-09. Orphan designation status: Positive.
First published: 2011-12-20. Last updated: 2019-03-18.

Intended use: Treatment of sickle cell disease

Source: https://www.ema.europa.eu/en/medicines/human/orphan-designations/eu-3-11-936